EMA orphan designation EU/3/24/2933
Active substance: human IgG1 monoclonal antibody against hepatitis B virus, surface antigen
Designated 2024-06-28. Orphan designation status: Positive.
First published: 2024-08-05.

Intended use: Treatment of hepatitis D virus infection

Source: https://www.ema.europa.eu/en/medicines/human/orphan-designations/eu-3-24-2933